EMA orphan designation EU/3/16/1648
Active substance: Human/murine chimeric monoclonal antibody against endoglin (carotuximab)
Designated 2016-04-28. Orphan designation status: Withdrawn.
First published: 2016-05-30. Last updated: 2019-06-17.

Intended use: Treatment of soft tissue sarcoma

Source: https://www.ema.europa.eu/en/medicines/human/orphan-designations/eu-3-16-1648